EMA orphan designation EU/3/15/1564
Active substance: Dronabinol; cannabidiol
Designated 2015-10-09. Orphan designation status: Withdrawn.
First published: 2015-11-18. Last updated: 2015-11-18.

Intended use: Treatment of glioma

Source: https://www.ema.europa.eu/en/medicines/human/orphan-designations/eu-3-15-1564